EMA orphan designation EU/3/20/2254
Active substance: Combination of three adeno-associated viral vectors of serotype 8 containing the 5'-, the body- and the 3'- coding sequences of human CEP290 fused to inteins
Designated 2020-02-28. Orphan designation status: Positive.
First published: 2020-05-06. Last updated: 2022-11-07.

Intended use: Treatment of inherited retinal dystrophies

Source: https://www.ema.europa.eu/en/medicines/human/orphan-designations/eu-3-20-2254